EMA orphan designation EU/3/12/1026
Active substance: Hexasodium phytate
Designated 2012-07-17. Orphan designation status: Positive.
First published: 2012-08-31. Last updated: 2022-11-22.

Intended use: Treatment of calciphylaxis

Source: https://www.ema.europa.eu/en/medicines/human/orphan-designations/eu-3-12-1026